EMA orphan designation EU/3/24/2980
Active substance: adeno-associated virus vector serotype SAN011 encoding a microRNA against DMPK mRNA
Designated 2024-10-11. Orphan designation status: Positive.
First published: 2024-11-14.

Intended use: Treatment of dystrophic myotonia type 1

Source: https://www.ema.europa.eu/en/medicines/human/orphan-designations/eu-3-24-2980